EMA orphan designation EU/3/09/619
Active substance: allogeneic ex-vivo-expanded umbilical-cord blood cells
Designated 2009-02-27. Orphan designation status: Withdrawn.
First published: 2009-10-30. Last updated: 2015-05-08.

Intended use: Treatment of acute myeloid leukaemia

Source: https://www.ema.europa.eu/en/medicines/human/orphan-designations/eu-3-09-619